EMA orphan designation EU/3/18/2052
Designated 2018-07-31. Orphan designation status: Positive.
First published: 2018-10-11.

Intended use: Treatment of primary hyperoxaluria

Source: https://www.ema.europa.eu/en/medicines/human/orphan-designations/eu-3-18-2052